EMA orphan designation EU/3/22/2676
Active substance: Mazindol
Designated 2022-08-10. Orphan designation status: Positive.
First published: 2022-12-15.

Intended use: Treatment of idiopathic hypersomnia

Source: https://www.ema.europa.eu/en/medicines/human/orphan-designations/eu-3-22-2676